EMA orphan designation EU/3/07/506
Active substance: heterologous human adult liver-derived stem cells
Designated 2007-11-29. Orphan designation status: Positive.
First published: 2008-07-02. Last updated: 2024-10-15.

Intended use: Treatment of Crigler-Najjar syndrome

Source: https://www.ema.europa.eu/en/medicines/human/orphan-designations/eu-3-07-506